EMA orphan designation EU/3/16/1701: Loargys
Active substance: poly(oxy-1,2-ethanediyl), alpha-(carboxymethyl)-omega-methoxy-, amide with arginase 1 [cobalt cofactor] (synthetic human) (1:10), trimer (pegzilarginase)
Designated 2016-07-14. Orphan designation status: Positive. EMA product number: EMEA/H/C/005484.
First published: 2016-09-06. Last updated: 2022-11-18.

Intended use: Treatment of hyperargininaemia

Source: https://www.ema.europa.eu/en/medicines/human/orphan-designations/eu-3-16-1701